EMA orphan designation EU/3/11/881
Active substance: acadesine
Designated 2011-08-05. Orphan designation status: Positive.
First published: 2011-08-16. Last updated: 2013-07-31.

Intended use: Treatment of multiple myeloma

Source: https://www.ema.europa.eu/en/medicines/human/orphan-designations/eu-3-11-881